EMA orphan designation EU/3/20/2345: Filspari
Active substance: sparsentan
Designated 2020-10-19. Orphan designation status: Positive. EMA product number: EMEA/H/C/005783.
First published: 2021-02-16. Last updated: 2022-01-12.

Intended use: Treatment of primary IgA nephropathy

Source: https://www.ema.europa.eu/en/medicines/human/orphan-designations/eu-3-20-2345